EMA orphan designation EU/3/17/1918
Active substance: autologous ex-vivo-expanded peripheral polyclonal lymphocytes enriched in activated natural killer cells
Designated 2017-10-16. Orphan designation status: Positive.
First published: 2018-01-10. Last updated: 2018-01-10.

Intended use: Treatment of multiple myeloma

Source: https://www.ema.europa.eu/en/medicines/human/orphan-designations/eu-3-17-1918